EMA orphan designation EU/3/06/365
Active substance: temsirolimus
Designated 2006-04-06. Orphan designation status: Expired.
First published: 2009-04-02. Last updated: 2018-01-22.

Intended use: Treatment of renal-cell carcinoma

Source: https://www.ema.europa.eu/en/medicines/human/orphan-designations/eu-3-06-365